EMA orphan designation EU/3/04/219
Active substance: HLA-B27-derived peptide (amino acid 125-138)
Designated 2004-09-02. Orphan designation status: Positive.
First published: 2006-06-09. Last updated: 2013-10-17.

Intended use: Treatment of autoimmune uveitis

Source: https://www.ema.europa.eu/en/medicines/human/orphan-designations/eu-3-04-219